EMA orphan designation EU/3/22/2608
Active substance: adeno-associated virus serotype R100 containing the human RPGRorf15 gene isoform
Designated 2022-05-16. Orphan designation status: Positive.
First published: 2022-12-14.

Intended use: Treatment of inherited retinal dystrophies due to defects in the RPGR gene

Source: https://www.ema.europa.eu/en/medicines/human/orphan-designations/eu-3-22-2608